EMA orphan designation EU/3/16/1804
Active substance: pegylated recombinant human interleukin-10
Designated 2016-12-12. Orphan designation status: Positive.
First published: 2017-01-09. Last updated: 2018-10-23.

Intended use: Treatment of pancreatic cancer

Source: https://www.ema.europa.eu/en/medicines/human/orphan-designations/eu-3-16-1804